EMA orphan designation EU/3/14/1398
Active substance: genetically modified serotype 5/3 adenovirus coding for granulocyte macrophage colony-stimulating factor
Designated 2014-12-16. Orphan designation status: Withdrawn.
First published: 2015-02-26. Last updated: 2026-05-26.

Intended use: Treatment of malignant mesothelioma

Source: https://www.ema.europa.eu/en/medicines/human/orphan-designations/eu-3-14-1398